EMA orphan designation EU/3/08/558
Active substance: sapacitabine
Designated 2008-07-10. Orphan designation status: Positive.
First published: 2009-05-15. Last updated: 2009-05-15.

Intended use: Treatment of acute myeloid leukaemia

Source: https://www.ema.europa.eu/en/medicines/human/orphan-designations/eu-3-08-558